EMA orphan designation EU/3/20/2272: Rystiggo
Active substance: rozanolixizumab
Designated 2020-04-22. Orphan designation status: Positive. EMA product number: EMEA/H/C/005824.
First published: 2020-08-13.

Intended use: Treatment of myasthenia gravis

Source: https://www.ema.europa.eu/en/medicines/human/orphan-designations/eu-3-20-2272